EMA orphan designation EU/3/03/175
Active substance: recombinant antibody derivative against human CD19 and CD3 (blinatumomab)
Designated 2003-12-01. Orphan designation status: Withdrawn.
First published: 2004-11-05. Last updated: 2020-12-11.

Intended use: Treatment of mantle cell lymphoma

Source: https://www.ema.europa.eu/en/medicines/human/orphan-designations/eu-3-03-175